EMA orphan designation EU/3/14/1353: Namuscla
Active substance: Mexiletine hydrochloride
Designated 2014-11-19. Orphan designation status: Positive. EMA product number: EMEA/H/C/004584.
First published: 2015-01-15. Last updated: 2018-06-13.

Intended use: Treatment of myotonic disorders

Source: https://www.ema.europa.eu/en/medicines/human/orphan-designations/eu-3-14-1353